EMA orphan designation EU/3/24/2901
Active substance: andecaliximab
Designated 2024-02-19. Orphan designation status: Positive.
First published: 2024-04-11.

Intended use: Treatment of fibrodysplasia ossificans progressiva

Source: https://www.ema.europa.eu/en/medicines/human/orphan-designations/eu-3-24-2901